EMA orphan designation EU/3/24/3021
Active substance: camostat mesilate
Designated 2025-01-16. Orphan designation status: Positive.
First published: 2025-02-20.

Intended use: Treatment of chronic pancreatitis

Source: https://www.ema.europa.eu/en/medicines/human/orphan-designations/eu-3-24-3021